EMA orphan designation EU/3/15/1455
Active substance: Human plasma-derived alpha-1 proteinase inhibitor
Designated 2015-03-19. Orphan designation status: Positive.
First published: 2015-05-11. Last updated: 2019-05-27.

Intended use: Treatment of graft-versus-host disease

Source: https://www.ema.europa.eu/en/medicines/human/orphan-designations/eu-3-15-1455